EMA orphan designation EU/3/16/1819
Active substance: Humanised IgG1 monoclonal antibody against the receptor-binding site of human placental growth factor
Designated 2017-01-12. Orphan designation status: Positive.
First published: 2017-07-14. Last updated: 2017-07-14.

Intended use: Treatment of medulloblastoma

Source: https://www.ema.europa.eu/en/medicines/human/orphan-designations/eu-3-16-1819